EMA orphan designation EU/3/13/1233
Active substance: allogeneic bone-marrow-derived ex-vivo-expanded multipotent adult progenitor cells
Designated 2014-01-16. Orphan designation status: Positive.
First published: 2014-03-24. Last updated: 2014-03-24.

Intended use: Prevention of graft-versus-host disease

Source: https://www.ema.europa.eu/en/medicines/human/orphan-designations/eu-3-13-1233